EMA orphan designation EU/3/00/010
Active substance: anagrelide hydrochloride
Designated 2000-12-30. Orphan designation status: Expired.
First published: 2009-06-15. Last updated: 2017-02-08.

Intended use: Treatment of essential thrombocythaemia

Source: https://www.ema.europa.eu/en/medicines/human/orphan-designations/eu-3-00-010